EMA orphan designation EU/3/22/2638
Active substance: modified mRNA encoding human methylmalonyl-coenzyme A mutase containing a polymorphism at position 671
Designated 2022-06-21. Orphan designation status: Positive.
First published: 2022-09-22.

Intended use: Treatment of methylmalonic acidaemia

Source: https://www.ema.europa.eu/en/medicines/human/orphan-designations/eu-3-22-2638